EMA orphan designation EU/3/15/1573
Active substance: adeno-associated viral vector serotype 8 encoding the human ATP7B gene under the control of the human alpha-1 antitrypsin promoter
Designated 2015-11-11. Orphan designation status: Positive.
First published: 2016-01-06. Last updated: 2016-01-06.

Intended use: Treatment of Wilson's disease

Source: https://www.ema.europa.eu/en/medicines/human/orphan-designations/eu-3-15-1573